EMA orphan designation EU/3/07/442
Active substance: enzastaurin hydrochloride
Designated 2007-03-20. Orphan designation status: Withdrawn.
First published: 2008-07-10. Last updated: 2023-09-12.

Intended use: Treatment of diffuse large B-cell lymphoma

Source: https://www.ema.europa.eu/en/medicines/human/orphan-designations/eu-3-07-442